EMA orphan designation EU/3/22/2643
Active substance: Humanised IgG4 monoclonal antibody against active complement component 1, subcomponent s
Designated 2022-07-18. Orphan designation status: Positive.
First published: 2023-01-25. Last updated: 2023-07-18.

Intended use: Treatment of autoimmune haemolytic anaemia

Source: https://www.ema.europa.eu/en/medicines/human/orphan-designations/eu-3-22-2643